EMA orphan designation EU/3/15/1494
Active substance: Humanised anti-CD37 monoclonal antibody conjugated to maytansinoid DM1 (naratuximab emtansine)
Orphan designation status: Withdrawn.
First published: 2015-06-30. Last updated: 2022-07-19.

Intended use: Treatment of diffuse large B-cell lymphoma

Source: https://www.ema.europa.eu/en/medicines/human/orphan-designations/eu-3-15-1494